EMA orphan designation EU/3/16/1653
Active substance: resiquimod
Designated 2016-04-28. Orphan designation status: Positive.
First published: 2016-05-31. Last updated: 2022-01-13.

Intended use: Treatment of cutaneous T-cell lymphoma

Source: https://www.ema.europa.eu/en/medicines/human/orphan-designations/eu-3-16-1653